EMA orphan designation EU/3/20/2247
Active substance: pelabresib monohydrate
Orphan designation status: Positive.
First published: 2020-05-05. Last updated: 2025-03-12.

Intended use: Treatment of myelofibrosis

Source: https://www.ema.europa.eu/en/medicines/human/orphan-designations/eu-3-20-2247